EMA orphan designation EU/3/10/806
Active substance: Methylthioninium
Designated 2010-11-26. Orphan designation status: Positive.
First published: 2010-12-15. Last updated: 2019-03-28.

Intended use: treatment of progressive non-fluent aphasia

Source: https://www.ema.europa.eu/en/medicines/human/orphan-designations/eu-3-10-806